EMA orphan designation EU/3/10/783
Active substance: recombinant fusion protein consisting of human coagulation factor VIII attached to the Fc domain of human IgG1 (efmoroctocog alfa)
Designated 2010-09-20. Orphan designation status: Withdrawn.
First published: 2010-10-18. Last updated: 2018-02-12.

Intended use: Treatment of haemophilia A

Source: https://www.ema.europa.eu/en/medicines/human/orphan-designations/eu-3-10-783